EMA orphan designation EU/3/22/2693
Active substance: crofelemer
Designated 2022-10-11. Orphan designation status: Positive.
First published: 2023-01-24.

Intended use: Treatment of microvillus inclusion disease

Source: https://www.ema.europa.eu/en/medicines/human/orphan-designations/eu-3-22-2693